EMA orphan designation EU/3/19/2152
Active substance: 3-(3-(3,5-bis(trifluoromethyl)phenyl)-1h-pyrazol-1-yl)propanoic acid
Designated 2019-04-24. Orphan designation status: Withdrawn.
First published: 2019-06-14. Last updated: 2023-04-13.

Intended use: Treatment of Stargardt's disease

Source: https://www.ema.europa.eu/en/medicines/human/orphan-designations/eu-3-19-2152